EMA orphan designation EU/3/13/1168
Active substance: ex-vivo-expanded autologous human corneal epithelium containing stem cells
Designated 2013-07-17. Orphan designation status: Positive.
First published: 2013-08-16. Last updated: 2013-08-16.

Intended use: Treatment of limbal stem cell deficiency

Source: https://www.ema.europa.eu/en/medicines/human/orphan-designations/eu-3-13-1168